EMA orphan designation EU/3/06/419
Active substance: paclitaxel
Designated 2006-10-31. Orphan designation status: Positive.
First published: 2009-04-02. Last updated: 2016-08-16.

Intended use: Treatment of pancreatic cancer

Source: https://www.ema.europa.eu/en/medicines/human/orphan-designations/eu-3-06-419